EMA orphan designation EU/3/13/1216: Livmarli
Active substance: (4R,5R)-1-[[4-[[4-[3,3-dibutyl-7-(dimethylamino)-2,3,4,5- tetrahydro-4-hydroxy-1,1-dioxido-1-benzothiepin-5-yl]phenoxy]methyl]phenyl]methyl]-4-aza-1-azoniabicyclo[2.2.2]octane chloride (maralixibat chloride)
Designated 2014-01-16. Orphan designation status: Positive. EMA product number: EMEA/H/C/005551.
First published: 2014-01-22. Last updated: 2021-12-03.

Intended use: Treatment of progressive familial intrahepatic cholestasis

Source: https://www.ema.europa.eu/en/medicines/human/orphan-designations/eu-3-13-1216